EMA orphan designation EU/3/16/1659
Active substance: arimoclomol citrate
Orphan designation status: Withdrawn.
First published: 2016-06-28. Last updated: 2022-07-19.

Intended use: Treatment of inclusion body myositis

Source: https://www.ema.europa.eu/en/medicines/human/orphan-designations/eu-3-16-1659